EMA orphan designation EU/3/18/2085
Active substance: autologous human adipose perivascular stromal cells genetically modified to secrete soluble tumour necrosis factor-related apoptosis-inducing ligand
Designated 2018-11-19. Orphan designation status: Positive.
First published: 2019-02-20.

Intended use: Treatment of pancreatic cancer

Source: https://www.ema.europa.eu/en/medicines/human/orphan-designations/eu-3-18-2085